EMA orphan designation EU/3/14/1306
Active substance: cysteamine bitartrate (gastroresistant) (mercaptamine)
Designated 2014-07-29. Orphan designation status: Positive.
First published: 2014-09-01. Last updated: 2014-09-01.

Intended use: Treatment of Huntington’s disease

Source: https://www.ema.europa.eu/en/medicines/human/orphan-designations/eu-3-14-1306